EMA orphan designation EU/3/20/2299
Active substance: C-type natriuretic peptide conjugated to multi-arm polyethylene glycol carrier through a cleavable linker
Designated 2020-07-27. Orphan designation status: Positive.
First published: 2020-11-19.

Intended use: Treatment of achondroplasia

Source: https://www.ema.europa.eu/en/medicines/human/orphan-designations/eu-3-20-2299